EMA orphan designation EU/3/01/072
Active substance: apomorphine
Designated 2001-12-05. Orphan designation status: Withdrawn.
First published: 2009-03-03. Last updated: 2009-03-03.

Intended use: Treatment of off-periods in Parkinson's disease not responding to other oral treatment

Source: https://www.ema.europa.eu/en/medicines/human/orphan-designations/eu-3-01-072